EMA orphan designation EU/3/20/2317
Active substance: allogeneic T-cell precursors, mobilised peripheral blood-derived, ex vivo cultured
Designated 2020-08-21. Orphan designation status: Positive.
First published: 2020-11-25.

Intended use: Treatment in haematopoietic stem cell transplantation

Source: https://www.ema.europa.eu/en/medicines/human/orphan-designations/eu-3-20-2317